EMA orphan designation EU/3/15/1598
Active substance: 2-(2-Chlorobenzylidene)hydrazinecarboximidamide acetate
Designated 2015-12-14. Orphan designation status: Positive.
First published: 2016-02-03. Last updated: 2016-02-03.

Intended use: Treatment of Charcot-Marie-Tooth disease

Source: https://www.ema.europa.eu/en/medicines/human/orphan-designations/eu-3-15-1598